EMA orphan designation EU/3/11/946
Active substance: recombinant homodimer of the human annexin V
Designated 2012-01-11. Orphan designation status: Withdrawn.
First published: 2012-02-01. Last updated: 2014-04-01.

Intended use: Prevention of ischaemia/reperfusion injury associated with solid organ transplantation

Source: https://www.ema.europa.eu/en/medicines/human/orphan-designations/eu-3-11-946